EMA orphan designation EU/3/25/3111
Active substance: pegtarazimod
Designated 2025-08-22. Orphan designation status: Positive.
First published: 2026-04-10.

Intended use: Treatment of graft-versus-host disease

Source: https://www.ema.europa.eu/en/medicines/human/orphan-designations/eu-3-25-3111